EMA orphan designation EU/3/15/1475
Active substance: 1-(4-(N-glycylamido)phenyl)-3-trifluoromethyl-5-(phenanthren-2-yl)-pyrazole-hydrochloride
Designated 2015-04-24. Orphan designation status: Positive.
First published: 2015-05-21. Last updated: 2015-05-21.

Intended use: Treatment of cryptococcosis

Source: https://www.ema.europa.eu/en/medicines/human/orphan-designations/eu-3-15-1475